EMA orphan designation EU/3/23/2836
Active substance: Humanised IgG4 monoclonal antibody against C1q
Orphan designation status: Positive.
First published: 2024-01-24. Last updated: 2025-03-12.

Intended use: Treatment of Guillain-Barré syndrome

Source: https://www.ema.europa.eu/en/medicines/human/orphan-designations/eu-3-23-2836